EMA orphan designation EU/3/21/2431
Active substance: human IgG1 monoclonal antibody against alpha-synuclein
Designated 2021-05-20. Orphan designation status: Positive.
First published: 2022-03-17.

Intended use: Treatment of multiple system atrophy

Source: https://www.ema.europa.eu/en/medicines/human/orphan-designations/eu-3-21-2431